EMA orphan designation EU/3/17/1898
Active substance: Adeno-associated viral vector serotype Anc80 containing the truncated human ATP7B gene under the control of the human alpha-1 antitrypsin promoter
Designated 2017-08-23. Orphan designation status: Withdrawn.
First published: 2017-10-17. Last updated: 2020-12-03.

Intended use: Treatment of Wilson's disease

Source: https://www.ema.europa.eu/en/medicines/human/orphan-designations/eu-3-17-1898